EMA orphan designation EU/3/22/2719
Active substance: adeno-associated viral vector serotype 9 containing the human ABCD1 gene
Designated 2022-11-11. Orphan designation status: Withdrawn.
First published: 2023-01-25.

Intended use: Treatment of adrenoleukodystrophy

Source: https://www.ema.europa.eu/en/medicines/human/orphan-designations/eu-3-22-2719